EMA orphan designation EU/3/18/2083
Active substance: allogeneic faecal microbiota, pooled
Designated 2018-11-19. Orphan designation status: Positive.
First published: 2019-02-25. Last updated: 2022-09-15.

Intended use: Treatment of graft-versus-host disease

Source: https://www.ema.europa.eu/en/medicines/human/orphan-designations/eu-3-18-2083